EMA orphan designation EU/3/25/3175
Active substance: iniparib
Designated 2025-09-12. Orphan designation status: Positive.
First published: 2026-03-26.

Intended use: Treatment of glioma

Source: https://www.ema.europa.eu/en/medicines/human/orphan-designations/eu-3-25-3175